EMA orphan designation EU/3/02/114
Active substance: myristolated-peptidyl-recombinant human CD59
Designated 2002-09-11. Orphan designation status: Withdrawn.
First published: 2009-09-23. Last updated: 2009-09-23.

Intended use: Treatment of paroxysmal nocturnal haemoglobinuria

Source: https://www.ema.europa.eu/en/medicines/human/orphan-designations/eu-3-02-114